EMA orphan designation EU/3/26/3198
Active substance: orziloben
Orphan designation status: Positive.
First published: 2026-04-14.

Intended use: Treatment of intestinal failure-associated liver disease

Source: https://www.ema.europa.eu/en/medicines/human/orphan-designations/eu-3-26-3198